EMA orphan designation EU/3/16/1616
Active substance: diclofenamide
Designated 2016-02-17. Orphan designation status: Positive.
First published: 2016-04-07. Last updated: 2021-04-26.

Intended use: Treatment of periodic paralysis

Source: https://www.ema.europa.eu/en/medicines/human/orphan-designations/eu-3-16-1616